EMA orphan designation EU/3/18/2116: Kaftrio
Active substance: Ivacaftor, N-(1,3-dimethyl-1H-pyrazole-4-sulfonyl)-6-[3-(3,3,3-trifluoro-2,2-dimethylpropoxy)-1H-pyrazol-1-yl]-2-[(4S)-2,2,4-trimethylpyrrolidin-1-yl]pyridine-3-carboxamide, tezacaftor (ivacaftor / tezacaftor / elexacaftor)
Designated 2018-12-14. Orphan designation status: Positive. EMA product number: EMEA/H/C/005269.
First published: 2019-03-07. Last updated: 2022-01-25.

Intended use: Treatment of cystic fibrosis

Source: https://www.ema.europa.eu/en/medicines/human/orphan-designations/eu-3-18-2116